EMA orphan designation EU/3/25/3189
Active substance: sirolimus
Designated 2026-01-09. Orphan designation status: Positive.
First published: 2026-04-10.

Intended use: Treatment of osteosarcoma

Source: https://www.ema.europa.eu/en/medicines/human/orphan-designations/eu-3-25-3189